EMA orphan designation EU/3/11/948
Active substance: sodium phenylbutyrate
Designated 2012-01-11. Orphan designation status: Withdrawn.
First published: 2012-02-01. Last updated: 2017-07-17.

Intended use: Treatment of 5q spinal muscular atrophy

Source: https://www.ema.europa.eu/en/medicines/human/orphan-designations/eu-3-11-948